EMA orphan designation EU/3/18/2100
Active substance: propagermanium
Designated 2018-11-19. Orphan designation status: Withdrawn.
First published: 2019-02-19. Last updated: 2025-01-15.

Intended use: Treatment of focal segmental glomerulosclerosis

Source: https://www.ema.europa.eu/en/medicines/human/orphan-designations/eu-3-18-2100